EMA orphan designation EU/3/16/1763
Active substance: tadekinig alfa
Designated 2016-10-14. Orphan designation status: Positive.
First published: 2016-11-15. Last updated: 2021-02-19.

Intended use: Treatment of haemophagocytic lymphohistiocytosis

Source: https://www.ema.europa.eu/en/medicines/human/orphan-designations/eu-3-16-1763